EMA orphan designation EU/3/10/729
Designated 2010-03-23. Orphan designation status: Withdrawn.
First published: 2010-03-26. Last updated: 2011-11-18.

Intended use: Treatment of acute myeloid leukaemia

Source: https://www.ema.europa.eu/en/medicines/human/orphan-designations/eu-3-10-729